EMA orphan designation EU/3/03/144
Active substance: Liarozole
Designated 2003-06-10. Orphan designation status: Withdrawn.
First published: 2003-09-26. Last updated: 2016-08-10.

Intended use: Treatment of congenital ichthyoses

Source: https://www.ema.europa.eu/en/medicines/human/orphan-designations/eu-3-03-144